EMA orphan designation EU/3/14/1295
Active substance: Marizomib
Designated 2014-07-29. Orphan designation status: Positive.
First published: 2014-09-01. Last updated: 2018-02-13.

Intended use: Treatment of plasma cell myeloma

Source: https://www.ema.europa.eu/en/medicines/human/orphan-designations/eu-3-14-1295